EMA orphan designation EU/3/16/1611
Active substance: ex-vivo-expanded autologous fibroblasts transduced with lentiviral vector containing the COL7A1 gene
Designated 2016-02-17. Orphan designation status: Positive.
First published: 2016-04-06. Last updated: 2016-04-06.

Intended use: Treatment of epidermolysis bullosa

Source: https://www.ema.europa.eu/en/medicines/human/orphan-designations/eu-3-16-1611